EMA orphan designation EU/3/16/1780
Active substance: Ibrutinib
Designated 2016-11-18. Orphan designation status: Withdrawn.
First published: 2016-12-14. Last updated: 2021-11-23.

Intended use: Treatment of graft-versus-host disease

Source: https://www.ema.europa.eu/en/medicines/human/orphan-designations/eu-3-16-1780